EMA orphan designation EU/3/09/645
Active substance: octreotide hydrochloride
Designated 2009-06-12. Orphan designation status: Withdrawn.
First published: 2009-09-17. Last updated: 2025-06-30.

Intended use: Treatment of acromegaly

Source: https://www.ema.europa.eu/en/medicines/human/orphan-designations/eu-3-09-645